EMA orphan designation EU/3/01/023
Active substance: pegvisomant
Designated 2001-02-15. Orphan designation status: Expired.
First published: 2005-11-29. Last updated: 2013-02-27.

Intended use: Treatment of acromegaly

Source: https://www.ema.europa.eu/en/medicines/human/orphan-designations/eu-3-01-023